EMA orphan designation EU/3/21/2449
Active substance: imatinib
Designated 2021-06-21. Orphan designation status: Positive.
First published: 2022-05-11. Last updated: 2024-04-08.

Intended use: Treatment of pulmonary arterial hypertension

Source: https://www.ema.europa.eu/en/medicines/human/orphan-designations/eu-3-21-2449